EMA orphan designation EU/3/23/2825
Active substance: cedazuridine; decitabine
Designated 2023-08-16. Orphan designation status: Withdrawn.
First published: 2024-02-01. Last updated: 2026-05-26.

Intended use: Treatment of myelodysplastic syndromes

Source: https://www.ema.europa.eu/en/medicines/human/orphan-designations/eu-3-23-2825